EMA orphan designation EU/3/25/3131
Active substance: sonpiretigene isteparvovec
Designated 2025-08-22. Orphan designation status: Positive.
First published: 2026-04-10.

Intended use: Treatment of non-syndromic inherited retinal dystrophies of the rod-dominant phenotype

Source: https://www.ema.europa.eu/en/medicines/human/orphan-designations/eu-3-25-3131